EMA orphan designation EU/3/17/1857
Active substance: Ketoconazole
Designated 2017-03-20. Orphan designation status: Positive.
First published: 2017-05-05. Last updated: 2017-05-05.

Intended use: Treatment of granulosa cell tumours

Source: https://www.ema.europa.eu/en/medicines/human/orphan-designations/eu-3-17-1857